EMA orphan designation EU/3/07/518
Active substance: Methyl 4,6-diamino-2-[1-(2-fluorobenzyl)-1H-pyrazolo[3,4-b]pyridine-3-yl]-5-pyrimidinyl(methyl)carbamate (riociguat)
Designated 2007-12-20. Orphan designation status: Withdrawn.
First published: 2009-09-23. Last updated: 2022-06-14.

Intended use: Treatment of pulmonary arterial hypertension

Source: https://www.ema.europa.eu/en/medicines/human/orphan-designations/eu-3-07-518